EMA orphan designation EU/3/24/2916
Active substance: quabodepistat
Designated 2024-05-24. Orphan designation status: Positive.
First published: 2024-08-22.

Intended use: Treatment of tuberculosis

Source: https://www.ema.europa.eu/en/medicines/human/orphan-designations/eu-3-24-2916